EMA orphan designation EU/3/24/2941
Active substance: Ethyl(2E,4S)-4-({(2S)-2-[3-{[(5-methyl-1,2-oxazol-3-yl)carbonyl]amino}-2-oxopyridin-1(2H)-yl]pent-4-ynoyl}amino)-5-[(3S)-2-oxopyrrolidin-3-yl]pent-2-enoate, Pocapavir
Designated 2024-06-28. Orphan designation status: Positive.
First published: 2024-08-22.

Intended use: Treatment of poliovirus infection

Source: https://www.ema.europa.eu/en/medicines/human/orphan-designations/eu-3-24-2941